EMA orphan designation EU/3/05/336
Active substance: brostallicin
Designated 2005-12-23. Orphan designation status: Withdrawn.
First published: 2009-09-17. Last updated: 2013-07-08.

Intended use: Treatment of soft tissue sarcoma

Source: https://www.ema.europa.eu/en/medicines/human/orphan-designations/eu-3-05-336